EMA orphan designation EU/3/21/2458: Ziihera
Active substance: zanidatamab
Designated 2021-07-19. Orphan designation status: Positive. EMA product number: EMEA/H/C/006380.
First published: 2021-11-12. Last updated: 2025-04-25.

Intended use: Treatment of biliary tract cancer

Source: https://www.ema.europa.eu/en/medicines/human/orphan-designations/eu-3-21-2458